EMA orphan designation EU/3/11/943
Active substance: mogamulizumab
Designated 2012-01-11. Orphan designation status: Positive.
First published: 2012-02-01. Last updated: 2018-10-18.

Intended use: Treatment of peripheral T-cell lymphoma (nodal, other extranodal and leukaemic/disseminated)

Source: https://www.ema.europa.eu/en/medicines/human/orphan-designations/eu-3-11-943